EMA orphan designation EU/3/22/2721
Active substance: N-(4-(4-amino-5-(3-fluoro-4-((4-methylpyrimidin-2-yl)oxy)phenyl)-7-methyl-7H-pyrrolo[2,3-d] pyrimidin-6-yl)phenyl)methacrylamide hydrochloride
Designated 2022-11-10. Orphan designation status: Positive.
First published: 2023-01-23. Last updated: 2025-02-24.

Intended use: Treatment of biliary tract cancer

Source: https://www.ema.europa.eu/en/medicines/human/orphan-designations/eu-3-22-2721